EMA orphan designation EU/3/00/011
Active substance: busulfan
Designated 2000-12-30. Orphan designation status: Expired.
First published: 2009-05-15. Last updated: 2013-07-31.

Intended use: Conditioning treatment prior to haematopoietic-progenitor-cell transplantation

Source: https://www.ema.europa.eu/en/medicines/human/orphan-designations/eu-3-00-011